EMA orphan designation EU/3/14/1273
Active substance: autologous dendritic cells pulsed with RNA from glioma stem cells
Designated 2014-06-04. Orphan designation status: Positive.
First published: 2014-07-10. Last updated: 2014-07-10.

Intended use: Treatment of glioma

Source: https://www.ema.europa.eu/en/medicines/human/orphan-designations/eu-3-14-1273